EMA orphan designation EU/3/12/1033
Active substance: N-butyldeoxygalactonojirimycin
Designated 2012-08-09. Orphan designation status: Positive.
First published: 2012-09-27. Last updated: 2015-03-24.

Intended use: Treatment of Fabry disease

Source: https://www.ema.europa.eu/en/medicines/human/orphan-designations/eu-3-12-1033